EMA orphan designation EU/3/19/2156
Active substance: modified messenger ribonucleic acid encoding human propionyl-coenzyme A carboxylase alpha and beta subunits encapsulated into lipid nanoparticle
Designated 2019-04-24. Orphan designation status: Positive.
First published: 2019-06-14. Last updated: 2022-06-22.

Intended use: Treatment of propionic acidaemia

Source: https://www.ema.europa.eu/en/medicines/human/orphan-designations/eu-3-19-2156